EMA orphan designation EU/3/12/1097
Active substance: lenalidomide
Designated 2013-01-24. Orphan designation status: Withdrawn.
First published: 2013-03-01. Last updated: 2020-02-11.

Intended use: Treatment of follicular lymphoma

Source: https://www.ema.europa.eu/en/medicines/human/orphan-designations/eu-3-12-1097